EMA orphan designation EU/3/05/273
Active substance: ambrisentan
Designated 2005-04-11. Orphan designation status: Withdrawn.
First published: 2008-07-29. Last updated: 2018-07-18.

Intended use: Treatment of pulmonary arterial hypertension and chronic thromboembolic pulmonary hypertension

Source: https://www.ema.europa.eu/en/medicines/human/orphan-designations/eu-3-05-273